EMA orphan designation EU/3/08/602
Active substance: Milatuzumab
Designated 2009-01-19. Orphan designation status: Withdrawn.
First published: 2009-06-29. Last updated: 2020-02-18.

Intended use: Treatment of chronic lymphocytic leukaemia

Source: https://www.ema.europa.eu/en/medicines/human/orphan-designations/eu-3-08-602